EMA orphan designation EU/3/15/1491
Active substance: adeno-associated viral vector serotype 9 containing the human HGSNAT gene
Designated 2015-05-21. Orphan designation status: Positive.
First published: 2015-06-30. Last updated: 2019-05-21.

Intended use: Treatment of mucopolysaccharidosis IIIC (Sanfilippo C syndrome)

Source: https://www.ema.europa.eu/en/medicines/human/orphan-designations/eu-3-15-1491